EMA orphan designation EU/3/23/2858
Active substance: Elesclomol-copper
Orphan designation status: Positive.
First published: 2025-07-25.

Intended use: Treatment of Menkes disease

Source: https://www.ema.europa.eu/en/medicines/human/orphan-designations/eu-3-23-2858-0